EMA orphan designation EU/3/18/2094
Active substance: anti-GD2 monoclonal antibody 3F8 humanised
Designated 2018-11-19. Orphan designation status: Positive.
First published: 2019-02-25.

Intended use: Treatment of neuroblastoma

Source: https://www.ema.europa.eu/en/medicines/human/orphan-designations/eu-3-18-2094